EMA orphan designation EU/3/10/738: Ravicti
Active substance: Glyceryl tri-(4-phenylbutyrate)
Designated 2010-06-10. Orphan designation status: Positive. EMA product number: EMEA/H/C/003822.
First published: 2010-06-24. Last updated: 2022-11-18.

Intended use: Ornithine translocase deficiency (hyperornithinaemia-hyperammonaemia homocitrullinuria (HHH) syndrom

Source: https://www.ema.europa.eu/en/medicines/human/orphan-designations/eu-3-10-738